EMA orphan designation EU/3/15/1543
Active substance: (S)-6-hydroxy-2,5,7,8-tetramethyl-N-((R)-piperidin-3-yl)chroman-2-carboxamide hydrochloride
Designated 2015-08-10. Orphan designation status: Withdrawn.
First published: 2015-10-02. Last updated: 2023-03-27.

Intended use: Treatment of mitochondrial encephalomyopathy, lactic acidosis and stroke-like episodes

Source: https://www.ema.europa.eu/en/medicines/human/orphan-designations/eu-3-15-1543